EMA orphan designation EU/3/21/2445
Active substance: L-ergothioneine
Designated 2021-06-21. Orphan designation status: Positive.
First published: 2022-05-11.

Intended use: Treatment of cystinuria

Source: https://www.ema.europa.eu/en/medicines/human/orphan-designations/eu-3-21-2445